EMA orphan designation EU/3/18/2048
Active substance: N-acetylgalactosamine-conjugated synthetic double-stranded oligomer specific to serpin family A member 1 gene
Designated 2018-07-31. Orphan designation status: Positive.
First published: 2018-10-11. Last updated: 2021-03-02.

Intended use: Treatment of congenital alpha-1 antitrypsin deficiency

Source: https://www.ema.europa.eu/en/medicines/human/orphan-designations/eu-3-18-2048